EMA orphan designation EU/3/12/952
Active substance: nimorazole maleate
Designated 2012-02-09. Orphan designation status: Withdrawn.
First published: 2012-03-01. Last updated: 2021-03-19.

Intended use: Treatment of squamous-cell carcinoma of the head and neck in patients undergoing radiotherapy

Source: https://www.ema.europa.eu/en/medicines/human/orphan-designations/eu-3-12-952